EMA orphan designation EU/3/21/2403
Active substance: rintatolimod
Designated 2022-02-19. Orphan designation status: Positive.
First published: 2022-02-22.

Intended use: Treatment of pancreatic cancer

Source: https://www.ema.europa.eu/en/medicines/human/orphan-designations/eu-3-21-2403